EMA orphan designation EU/3/24/3006
Active substance: adeno-associated virus serotype 5 containing the human RORA gene
Designated 2024-12-13. Orphan designation status: Positive.
First published: 2025-01-13.

Intended use: Treatment of inherited retinal dystrophies due to dysfunction in the ABCA4 gene

Source: https://www.ema.europa.eu/en/medicines/human/orphan-designations/eu-3-24-3006